EMA orphan designation EU/3/19/2230
Active substance: efgartigimod alfa
Designated 2019-12-16. Orphan designation status: Positive.
First published: 2020-02-25. Last updated: 2021-09-02.

Intended use: Treatment of immune thrombocytopenia

Source: https://www.ema.europa.eu/en/medicines/human/orphan-designations/eu-3-19-2230